EMA orphan designation EU/3/01/033
Active substance: ranpirnase
Designated 2001-03-29. Orphan designation status: Withdrawn.
First published: 2009-03-03. Last updated: 2010-11-19.

Intended use: Treatment of malignant mesothelioma

Source: https://www.ema.europa.eu/en/medicines/human/orphan-designations/eu-3-01-033